EMA orphan designation EU/3/10/755
Active substance: octenidine dihydrochloride
Designated 2010-07-27. Orphan designation status: Positive.
First published: 2010-08-05. Last updated: 2010-08-05.

Intended use: Prevention of late-onset sepsis in premature infants of ≤ to 32 weeks of gestational age

Source: https://www.ema.europa.eu/en/medicines/human/orphan-designations/eu-3-10-755